EMA orphan designation EU/3/23/2786
Active substance: nicotinamide mononucleotide
Designated 2023-06-20. Orphan designation status: Positive.
First published: 2024-01-30.

Intended use: Treatment of sickle cell disease

Source: https://www.ema.europa.eu/en/medicines/human/orphan-designations/eu-3-23-2786